EMA orphan designation EU/3/19/2151
Active substance: Marzeptacog alfa (activated)
Designated 2019-04-01. Orphan designation status: Withdrawn.
First published: 2019-05-07. Last updated: 2026-05-26.

Intended use: Treatment of haemophilia B

Source: https://www.ema.europa.eu/en/medicines/human/orphan-designations/eu-3-19-2151